EMA orphan designation EU/3/21/2409
Active substance: Dodecyl creatine ester, dodecyl creatine ester hydrochloride
Designated 2021-02-19. Orphan designation status: Positive.
First published: 2022-02-23. Last updated: 2023-02-06.

Intended use: Treatment of creatine deficiency syndromes

Source: https://www.ema.europa.eu/en/medicines/human/orphan-designations/eu-3-21-2409